EMA orphan designation EU/3/17/1949
Active substance: acetylleucine
Designated 2017-12-12. Orphan designation status: Positive.
First published: 2018-01-24. Last updated: 2019-04-25.

Intended use: Treatment of GM2 gangliosidosis

Source: https://www.ema.europa.eu/en/medicines/human/orphan-designations/eu-3-17-1949